EMA orphan designation EU/3/12/1034
Active substance: Humanised monoclonal antibody against P-selectin (crizanlizumab)
Designated 2012-08-09. Orphan designation status: Withdrawn.
First published: 2012-09-26. Last updated: 2026-05-26.

Intended use: Treatment of sickle cell disease

Source: https://www.ema.europa.eu/en/medicines/human/orphan-designations/eu-3-12-1034